EMA orphan designation EU/3/24/3011
Active substance: autologous T cells transduced with lentiviral vector containing a chimeric antigen receptor directed against CD19
Orphan designation status: Positive.
First published: 2025-01-13. Last updated: 2025-03-12.

Intended use: Treatment of myasthenia gravis

Source: https://www.ema.europa.eu/en/medicines/human/orphan-designations/eu-3-24-3011